EMA orphan designation EU/3/21/2567
Active substance: melatonin
Designated 2022-01-14. Orphan designation status: Positive.
First published: 2022-06-15.

Intended use: Treatment of pre-eclampsia

Source: https://www.ema.europa.eu/en/medicines/human/orphan-designations/eu-3-21-2567